EMA orphan designation EU/3/17/1891
Active substance: Bacillus subtilis oxalate decarboxylase
Designated 2017-07-17. Orphan designation status: Positive.
First published: 2017-08-15. Last updated: 2017-08-15.

Intended use: Treatment of primary hyperoxaluria

Source: https://www.ema.europa.eu/en/medicines/human/orphan-designations/eu-3-17-1891